EMA orphan designation EU/3/06/396
Active substance: cardiotrophin-1
Designated 2006-08-28. Orphan designation status: Positive.
First published: 2009-04-24. Last updated: 2010-06-23.

Intended use: Prevention of the ischemia/reperfusion injury associated with solid organ transplantation

Source: https://www.ema.europa.eu/en/medicines/human/orphan-designations/eu-3-06-396